EMA orphan designation EU/3/15/1431
Active substance: 3-[2-(4-carbamimidoyl-phenylcarbamoyl)-5-methoxy-4-vinyl-phenyl]-6-(cyclopropylmethyl-carbamoyl)-pyridine-2-carboxylic acid (avoralstat)
Designated 2015-02-12. Orphan designation status: Withdrawn.
First published: 2015-04-08. Last updated: 2017-04-25.

Intended use: Treatment of hereditary angioedema

Source: https://www.ema.europa.eu/en/medicines/human/orphan-designations/eu-3-15-1431